EMA orphan designation EU/3/23/2887
Active substance: zopapogene imadenovec
Designated 2024-01-12. Orphan designation status: Positive.
First published: 2024-04-11. Last updated: 2025-02-20.

Intended use: Treatment of recurrent respiratory papillomatosis

Source: https://www.ema.europa.eu/en/medicines/human/orphan-designations/eu-3-23-2887